EMA orphan designation EU/3/06/414
Active substance: catumaxomab
Designated 2006-11-03. Orphan designation status: Positive.
First published: 2009-02-24. Last updated: 2014-02-07.

Intended use: Treatment of gastric cancer

Source: https://www.ema.europa.eu/en/medicines/human/orphan-designations/eu-3-06-414